EMA orphan designation EU/3/24/2918
Active substance: autologous CD34+ cells transduced with a lentiviral vector containing the human RAG1 gene
Designated 2024-05-24. Orphan designation status: Positive.
First published: 2024-08-22.

Intended use: Treatment of recombination-activating gene 1 (RAG1) deficiency

Source: https://www.ema.europa.eu/en/medicines/human/orphan-designations/eu-3-24-2918